EMA orphan designation EU/3/15/1569
Active substance: pentetrazol
Designated 2015-11-11. Orphan designation status: Withdrawn.
First published: 2016-01-06. Last updated: 2020-12-10.

Intended use: Treatment of idiopathic hypersomnia

Source: https://www.ema.europa.eu/en/medicines/human/orphan-designations/eu-3-15-1569